EMA orphan designation EU/3/23/2848
Active substance: bortezomib
Designated 2023-11-08. Orphan designation status: Positive.
First published: 2024-02-01.

Intended use: Treatment of patients with light chain (AL) amyloidosis

Source: https://www.ema.europa.eu/en/medicines/human/orphan-designations/eu-3-23-2848